EMA orphan designation EU/3/25/3112
Active substance: lentiviral vector containing the human MMUT transgene
Designated 2025-08-22. Orphan designation status: Positive.
First published: 2026-04-10.

Intended use: Treatment of methylmalonic acidaemia

Source: https://www.ema.europa.eu/en/medicines/human/orphan-designations/eu-3-25-3112